EMA orphan designation EU/3/01/039
Active substance: Ecteinascidin 743 (trabectedin)
Designated 2001-05-30. Orphan designation status: Expired.
First published: 2007-12-04. Last updated: 2017-10-03.

Intended use: Treatment of soft tissue sarcoma

Source: https://www.ema.europa.eu/en/medicines/human/orphan-designations/eu-3-01-039